EMA orphan designation EU/3/06/405
Active substance: genetically modified allogenic (human) tumor cells for the expression of IL-7, GM-CSF, CD80 and CD154, in fixed combination with a DNA-based double stem loop immunomodulator (dSLIM)
Designated 2006-10-23. Orphan designation status: Positive.
First published: 2009-04-24. Last updated: 2009-04-24.

Intended use: Treatment of renal-cell carcinoma

Source: https://www.ema.europa.eu/en/medicines/human/orphan-designations/eu-3-06-405